EMA orphan designation EU/3/18/2006
Active substance: tazemetostat
Designated 2018-03-21. Orphan designation status: Withdrawn.
First published: 2018-05-17. Last updated: 2026-05-18.

Intended use: Treatment of malignant mesothelioma

Source: https://www.ema.europa.eu/en/medicines/human/orphan-designations/eu-3-18-2006